EMA orphan designation EU/3/17/1853
Active substance: antisense oligonucleotide targeting the USH2A gene
Designated 2017-03-20. Orphan designation status: Positive.
First published: 2017-05-05. Last updated: 2017-05-05.

Intended use: Treatment of retinitis pigmentosa

Source: https://www.ema.europa.eu/en/medicines/human/orphan-designations/eu-3-17-1853